EMA orphan designation EU/3/24/2960
Active substance: Interleukin 4 - interleukin 10 fusion protein
Orphan designation status: Positive.
First published: 2024-09-19.

Intended use: Treatment of complex regional pain syndrome

Source: https://www.ema.europa.eu/en/medicines/human/orphan-designations/eu-3-24-2960